EMA orphan designation EU/3/14/1350
Active substance: recombinant human monoclonal antibody of the IgG1 kappa class against human macrophage colony-stimulating factor (lacnotuzumab)
Designated 2014-10-15. Orphan designation status: Withdrawn.
First published: 2014-11-12. Last updated: 2026-05-26.

Intended use: Treatment of tenosynovial giant cell tumour, localised and diffuse type

Source: https://www.ema.europa.eu/en/medicines/human/orphan-designations/eu-3-14-1350